EMA orphan designation EU/3/13/1135
Active substance: recombinant human nerve growth factor
Designated 2013-06-07. Orphan designation status: Positive.
First published: 2013-06-25. Last updated: 2015-05-13.

Intended use: Treatment of retinitis pigmentosa

Source: https://www.ema.europa.eu/en/medicines/human/orphan-designations/eu-3-13-1135